EMA orphan designation EU/3/05/265
Active substance: ciclosporin
Designated 2005-03-10. Orphan designation status: Withdrawn.
First published: 2007-12-04. Last updated: 2015-03-23.

Intended use: Treatment of graft rejection after lung transplantation

Source: https://www.ema.europa.eu/en/medicines/human/orphan-designations/eu-3-05-265